EMA orphan designation EU/3/25/3129
Active substance: sonpiretigene isteparvovec
Designated 2025-08-22. Orphan designation status: Positive.
First published: 2026-04-10.

Intended use: Treatment of syndromic inherited retinal dystrophies of the rod-dominant phenotype

Source: https://www.ema.europa.eu/en/medicines/human/orphan-designations/eu-3-25-3129